EMA orphan designation EU/3/01/038
Active substance: retroviral gamma c cDNA containing vector
Designated 2001-05-30. Orphan designation status: Positive.
First published: 2009-05-15. Last updated: 2009-05-15.

Intended use: Treatment of severe combined immunodeficiency (SCID)-Xl Disease

Source: https://www.ema.europa.eu/en/medicines/human/orphan-designations/eu-3-01-038